EMA orphan designation EU/3/23/2762
Designated 2023-02-15. Orphan designation status: Positive.
First published: 2024-01-19.

Intended use: Treatment of GM2 gangliosidosis

Source: https://www.ema.europa.eu/en/medicines/human/orphan-designations/eu-3-23-2762